EMA orphan designation EU/3/12/1061
Active substance: tralokinumab
Designated 2012-11-08. Orphan designation status: Withdrawn.
First published: 2012-12-13. Last updated: 2016-10-21.

Intended use: Treatment of idiopathic pulmonary fibrosis

Source: https://www.ema.europa.eu/en/medicines/human/orphan-designations/eu-3-12-1061